EMA orphan designation EU/3/11/887: Omjjara
Active substance: N-(cyanomethyl)-4-(2-{[4-(morpholin-4-yl)phenyl]amino}pyrimidin-4-yl)benzamide, dihydrochloride salt (momelotinib)
Designated 2011-08-05. Orphan designation status: Positive. EMA product number: EMEA/H/C/005768.
First published: 2011-08-16. Last updated: 2022-10-04.

Intended use: Treatment of post-essential thrombocythaemia myelofibrosis

Source: https://www.ema.europa.eu/en/medicines/human/orphan-designations/eu-3-11-887